EMA orphan designation EU/3/16/1708: Prohippur
Active substance: sodium benzoate
Designated 2016-07-14. Orphan designation status: Positive. EMA product number: EMEA/H/C/004150.
First published: 2016-09-06. Last updated: 2016-09-06.

Intended use: Treatment of hyperargininaemia

Source: https://www.ema.europa.eu/en/medicines/human/orphan-designations/eu-3-16-1708